EMA orphan designation EU/3/07/432
Active substance: ex-vivo cultured adult human mesenchymal stem cells
Designated 2007-02-20. Orphan designation status: Positive.
First published: 2007-07-19. Last updated: 2021-10-13.

Intended use: Treatment of graft-versus-host disease

Source: https://www.ema.europa.eu/en/medicines/human/orphan-designations/eu-3-07-432